EMA orphan designation EU/3/03/156
Active substance: prasterone
Designated 2003-07-28. Orphan designation status: Positive.
First published: 2003-11-07. Last updated: 2003-11-07.

Intended use: Treatment of adrenal insufficiency

Source: https://www.ema.europa.eu/en/medicines/human/orphan-designations/eu-3-03-156